EMA orphan designation EU/3/14/1288
Active substance: Norursodeoxycholic acid
Designated 2014-07-04. Orphan designation status: Positive.
First published: 2014-07-16. Last updated: 2014-07-16.

Intended use: Treatment of primary sclerosing cholangitis

Source: https://www.ema.europa.eu/en/medicines/human/orphan-designations/eu-3-14-1288